EMA orphan designation EU/3/14/1250: Tegsedi
Active substance: phosphorothioate oligonucleotide targeted to transthyretin (inotersen)
Designated 2014-03-26. Orphan designation status: Positive. EMA product number: EMEA/H/C/004782.
First published: 2014-05-06. Last updated: 2019-05-17.

Intended use: Treatment of ATTR amyloidosis

Source: https://www.ema.europa.eu/en/medicines/human/orphan-designations/eu-3-14-1250